EMA orphan designation EU/3/10/726: Elelyso
Active substance: taliglucerase alfa
Designated 2010-03-23. Orphan designation status: Positive. EMA product number: EMEA/H/C/002250.
First published: 2010-03-26. Last updated: 2011-03-10.

Intended use: Treatment of Gaucher disease

Source: https://www.ema.europa.eu/en/medicines/human/orphan-designations/eu-3-10-726